EMA orphan designation EU/3/17/1937
Active substance: 4-amino-1-[(1S,4R,5S)-2-fluoro-4,5-dihydroxy-3-(hydroxymethyl)cyclopent-2-en-1-yl]pyrimidin-2-one
Designated 2017-10-12. Orphan designation status: Positive.
First published: 2018-01-15. Last updated: 2018-05-03.

Intended use: Treatment of pancreatic cancer

Source: https://www.ema.europa.eu/en/medicines/human/orphan-designations/eu-3-17-1937